EMA orphan designation EU/3/15/1571
Active substance: autologous T cells transduced with retroviral vector encoding an anti-CD19 CD28/CD3-zeta chimeric antigen receptor (axicabtagene ciloleucel)
Designated 2015-11-11. Orphan designation status: Withdrawn.
First published: 2016-01-06. Last updated: 2022-01-12.

Intended use: Treatment of acute lymphoblastic leukaemia

Source: https://www.ema.europa.eu/en/medicines/human/orphan-designations/eu-3-15-1571